EMA orphan designation EU/3/17/1896
Active substance: sirolimus
Designated 2017-07-17. Orphan designation status: Positive.
First published: 2017-08-14. Last updated: 2020-05-07.

Intended use: Treatment of pachyonychia congenita

Source: https://www.ema.europa.eu/en/medicines/human/orphan-designations/eu-3-17-1896